EMA orphan designation EU/3/15/1483
Active substance: xenon
Designated 2015-04-24. Orphan designation status: Positive.
First published: 2015-05-22. Last updated: 2015-05-22.

Intended use: Treatment of perinatal asphyxia

Source: https://www.ema.europa.eu/en/medicines/human/orphan-designations/eu-3-15-1483